EMA orphan designation EU/3/13/1122
Active substance: R,S-O-(3-piperidino-2-hydroxy-1-propyl)-nicotinic acid amidoxime dihydrochloride
Designated 2013-04-26. Orphan designation status: Positive.
First published: 2013-05-14. Last updated: 2015-03-24.

Intended use: Treatment of Duchenne muscular dystrophy

Source: https://www.ema.europa.eu/en/medicines/human/orphan-designations/eu-3-13-1122